EMA orphan designation EU/3/19/2145
Active substance: risdiplam
Designated 2019-02-26. Orphan designation status: Withdrawn.
First published: 2019-04-09. Last updated: 2023-09-12.

Intended use: Treatment of spinal muscular atrophy

Source: https://www.ema.europa.eu/en/medicines/human/orphan-designations/eu-3-19-2145